EMA orphan designation EU/3/18/2052
Active substance: synthetic double-stranded siRNA oligonucleotide directed against lactate dehydrogenase A mRNA and containing four modified nucleosides which form a ligand cluster of four N-acetylgalactosamine residues
Designated 2018-07-31. Orphan designation status: Positive.
First published: 2018-10-11. Last updated: 2023-02-24.

Intended use: Treatment of primary hyperoxaluria

Source: https://www.ema.europa.eu/en/medicines/human/orphan-designations/eu-3-18-2052-0